EMA orphan designation EU/3/11/947
Active substance: recombinant protein consisting of modified human growth hormone releasing hormone and the translocation and endopeptidase domains of botulinum toxin serotype D
Designated 2012-01-11. Orphan designation status: Withdrawn.
First published: 2012-02-01. Last updated: 2019-05-23.

Intended use: Treatment of acromegaly

Source: https://www.ema.europa.eu/en/medicines/human/orphan-designations/eu-3-11-947